EMA orphan designation EU/3/15/1536
Active substance: recombinant human acid ceramidase
Designated 2015-08-10. Orphan designation status: Positive.
First published: 2015-10-02. Last updated: 2021-06-25.

Intended use: Treatment of cystic fibrosis

Source: https://www.ema.europa.eu/en/medicines/human/orphan-designations/eu-3-15-1536